EMA orphan designation EU/3/02/102
Active substance: mitotane
Designated 2002-06-12. Orphan designation status: Expired.
First published: 2005-11-29. Last updated: 2014-06-19.

Intended use: Treatment of adrenal cortical carcinoma

Source: https://www.ema.europa.eu/en/medicines/human/orphan-designations/eu-3-02-102